EMA orphan designation EU/3/13/1203
Active substance: Ibrutinib
Designated 2013-11-13. Orphan designation status: Withdrawn.
First published: 2013-12-02. Last updated: 2021-11-23.

Intended use: Treatment of diffuse large B-cell lymphoma

Source: https://www.ema.europa.eu/en/medicines/human/orphan-designations/eu-3-13-1203